EMA orphan designation EU/3/09/665
Active substance: allogeneic ex-vivo-expanded umbilical-cord blood cells
Designated 2009-10-08. Orphan designation status: Withdrawn.
First published: 2009-10-20. Last updated: 2015-05-08.

Intended use: Treatment of chronic myeloid leukaemia

Source: https://www.ema.europa.eu/en/medicines/human/orphan-designations/eu-3-09-665